EMA orphan designation EU/3/17/1869
Active substance: rituximab
Designated 2017-04-20. Orphan designation status: Positive.
First published: 2017-05-15. Last updated: 2017-05-15.

Intended use: Treatment in solid organ transplantation

Source: https://www.ema.europa.eu/en/medicines/human/orphan-designations/eu-3-17-1869